EMA orphan designation EU/3/16/1736
Active substance: (6aR,10aR)-3-(1,1-dimethylheptyl)-delta8-tetrahydro-cannabinol-9-carboxylic acid
Designated 2016-10-14. Orphan designation status: Withdrawn.
First published: 2016-11-15. Last updated: 2024-04-09.

Intended use: Treatment of cystic fibrosis

Source: https://www.ema.europa.eu/en/medicines/human/orphan-designations/eu-3-16-1736